EMA orphan designation EU/3/13/1145
Active substance: genetically modified serotype 5/3 adenovirus coding for granulocyte-macrophage colony-stimulating factor
Designated 2013-06-19. Orphan designation status: Withdrawn.
First published: 2013-07-11. Last updated: 2026-05-26.

Intended use: Treatment of soft tissue sarcoma

Source: https://www.ema.europa.eu/en/medicines/human/orphan-designations/eu-3-13-1145